EMA orphan designation EU/3/16/1632
Active substance: Florilglutamic acid (18F)
Designated 2016-03-21. Orphan designation status: Withdrawn.
First published: 2016-05-02. Last updated: 2024-04-09.

Intended use: Diagnosis of hepatocellular carcinoma

Source: https://www.ema.europa.eu/en/medicines/human/orphan-designations/eu-3-16-1632